EMA orphan designation EU/3/07/468
Active substance: dihydroartemisinin; piperaquine
Designated 2007-08-03. Orphan designation status: Withdrawn.
First published: 2008-07-29. Last updated: 2011-09-05.

Intended use: Treatment of malaria

Source: https://www.ema.europa.eu/en/medicines/human/orphan-designations/eu-3-07-468